EMA orphan designation EU/3/12/1093
Active substance: 1,2:5,6-Dianhydrogalactitol
Designated 2013-01-24. Orphan designation status: Positive.
First published: 2013-03-01. Last updated: 2021-04-28.

Intended use: Treatment of glioma

Source: https://www.ema.europa.eu/en/medicines/human/orphan-designations/eu-3-12-1093